EMA orphan designation EU/3/13/1192
Active substance: zoledronic acid
Designated 2013-10-07. Orphan designation status: Positive.
First published: 2013-10-31. Last updated: 2013-10-31.

Intended use: Treatment of complex regional pain syndrome

Source: https://www.ema.europa.eu/en/medicines/human/orphan-designations/eu-3-13-1192